EMA orphan designation EU/3/15/1584
Active substance: variant of recombinant human fibroblast growth factor 19
Designated 2015-12-14. Orphan designation status: Withdrawn.
First published: 2016-02-02. Last updated: 2022-09-28.

Intended use: Treatment of primary sclerosing cholangitis

Source: https://www.ema.europa.eu/en/medicines/human/orphan-designations/eu-3-15-1584